EMA orphan designation EU/3/02/124
Active substance: 3,4-diaminopyridine phosphate (amifampridine)
Designated 2002-12-19. Orphan designation status: Withdrawn.
First published: 2008-08-18. Last updated: 2020-03-04.

Intended use: Treatment of Lambert-Eaton myasthenic syndrome

Source: https://www.ema.europa.eu/en/medicines/human/orphan-designations/eu-3-02-124